EMA orphan designation EU/3/21/2458
Active substance: zanidatamab
Orphan designation status: Positive.
First published: 2025-07-25.

Intended use: Treatment of biliary tract cancer

Source: https://www.ema.europa.eu/en/medicines/human/orphan-designations/eu-3-21-2458-0